EMA orphan designation EU/3/12/949
Active substance: sodium phenylbutyrate
Designated 2012-02-09. Orphan designation status: Withdrawn.
First published: 2012-03-01. Last updated: 2013-08-08.

Intended use: Treatment of citrullinaemia type 1

Source: https://www.ema.europa.eu/en/medicines/human/orphan-designations/eu-3-12-949